EMA orphan designation EU/3/05/297
Active substance: adeno-associated viral vector containing a modified U7 snRNA gene
Designated 2005-07-27. Orphan designation status: Withdrawn.
First published: 2005-10-11. Last updated: 2025-09-04.

Intended use: Treatment of Duchenne muscular dystrophy

Source: https://www.ema.europa.eu/en/medicines/human/orphan-designations/eu-3-05-297